EMA orphan designation EU/3/15/1480
Active substance: rintatolimod
Designated 2015-04-24. Orphan designation status: Positive.
First published: 2015-05-22. Last updated: 2015-05-22.

Intended use: Treatment of Ebola virus disease

Source: https://www.ema.europa.eu/en/medicines/human/orphan-designations/eu-3-15-1480